EMA orphan designation EU/3/06/383
Active substance: siplizumab
Designated 2006-06-29. Orphan designation status: Withdrawn.
First published: 2009-09-23. Last updated: 2013-04-09.

Intended use: Treatment of T-cell and NK-cell neoplasms

Source: https://www.ema.europa.eu/en/medicines/human/orphan-designations/eu-3-06-383